EMA orphan designation EU/3/23/2858
Active substance: Elesclomol-copper
Designated 2023-11-08. Orphan designation status: Positive.
First published: 2024-01-31.

Intended use: Treatment of Menkes disease

Source: https://www.ema.europa.eu/en/medicines/human/orphan-designations/eu-3-23-2858